EMA orphan designation EU/3/20/2307
Active substance: tinostamustine
Designated 2020-07-27. Orphan designation status: Positive.
First published: 2020-11-19.

Intended use: Treatment of T-cell prolymphocytic leukaemia

Source: https://www.ema.europa.eu/en/medicines/human/orphan-designations/eu-3-20-2307